EMA orphan designation EU/3/14/1421
Active substance: ponatinib hydrochloride
Designated 2015-01-15. Orphan designation status: Withdrawn.
First published: 2015-03-06. Last updated: 2022-03-18.

Intended use: Treatment of gastrointestinal stromal tumours

Source: https://www.ema.europa.eu/en/medicines/human/orphan-designations/eu-3-14-1421